EMA orphan designation EU/3/20/2283
Active substance: Ile-Ala-Leu-Ile-Leu-Glu-Pro-Ile-Cys-Cys-Gln-Glu-Arg-Ala-Ala-(discrete-polyethylene glycol)24
Designated 2020-06-04. Orphan designation status: Positive.
First published: 2020-08-19.

Intended use: Treatment of neonatal encephalopathy

Source: https://www.ema.europa.eu/en/medicines/human/orphan-designations/eu-3-20-2283